EMA orphan designation EU/3/17/1843
Active substance: soluble recombinant human fibroblast growth factor receptor 3
Designated 2017-02-27. Orphan designation status: Withdrawn.
First published: 2017-03-31. Last updated: 2023-03-24.

Intended use: Treatment of achondroplasia

Source: https://www.ema.europa.eu/en/medicines/human/orphan-designations/eu-3-17-1843